EMA orphan designation EU/3/12/1081
Active substance: triheptanoin
Designated 2012-12-06. Orphan designation status: Withdrawn.
First published: 2013-01-25. Last updated: 2016-08-12.

Intended use: Treatment of very-long-chain 3-hydroxyacyl-CoA-dehydrogenase deficiency

Source: https://www.ema.europa.eu/en/medicines/human/orphan-designations/eu-3-12-1081